EMA orphan designation EU/3/11/866
Active substance: allogeneic bone marrow stem cells treated ex vivo with 16,16-dimethyl prostaglandin E2
Designated 2011-05-13. Orphan designation status: Withdrawn.
First published: 2011-05-30. Last updated: 2017-02-07.

Intended use: Treatment of acute myeloid leukaemia

Source: https://www.ema.europa.eu/en/medicines/human/orphan-designations/eu-3-11-866